EMA orphan designation EU/3/05/285
Active substance: bimosiamose disodium
Designated 2005-05-27. Orphan designation status: Positive.
First published: 2005-06-29. Last updated: 2005-06-29.

Intended use: Treatment of acute lung Injury

Source: https://www.ema.europa.eu/en/medicines/human/orphan-designations/eu-3-05-285